EMA orphan designation EU/3/22/2679
Active substance: allogeneic placenta-derived decidual stromal cells
Designated 2022-08-10. Orphan designation status: Positive.
First published: 2022-12-15. Last updated: 2024-04-08.

Intended use: Treatment of graft-versus-host-disease

Source: https://www.ema.europa.eu/en/medicines/human/orphan-designations/eu-3-22-2679